EMA orphan designation EU/3/25/3127
Active substance: varegacestat
Designated 2025-08-22. Orphan designation status: Positive.
First published: 2026-04-10.

Intended use: Treatment of soft tissue sarcoma

Source: https://www.ema.europa.eu/en/medicines/human/orphan-designations/eu-3-25-3127